EMA orphan designation EU/3/14/1271
Active substance: 177Lu-tetraxetan-tetulomab
Designated 2014-06-04. Orphan designation status: Positive.
First published: 2014-07-10. Last updated: 2014-07-10.

Intended use: Treatment of follicular lymphoma

Source: https://www.ema.europa.eu/en/medicines/human/orphan-designations/eu-3-14-1271